EMA orphan designation EU/3/16/1822
Active substance: pentosan polysulfate sodium
Designated 2017-01-12. Orphan designation status: Positive.
First published: 2017-07-11. Last updated: 2017-07-11.

Intended use: Treatment of interstitial cystitis

Source: https://www.ema.europa.eu/en/medicines/human/orphan-designations/eu-3-16-1822